EMA orphan designation EU/3/17/1877
Active substance: tamoxifen citrate
Designated 2017-05-22. Orphan designation status: Positive.
First published: 2017-06-29. Last updated: 2017-06-29.

Intended use: Treatment of cystic fibrosis

Source: https://www.ema.europa.eu/en/medicines/human/orphan-designations/eu-3-17-1877